EMA orphan designation EU/3/25/3173
Active substance: cyclo(arginyl-glycyl-aspartyl-D-tyrosyl-lysyl)-nizaracianine-1
Designated 2025-09-12. Orphan designation status: Positive.
First published: 2026-03-26.

Intended use: Diagnosis of pancreatic cancer

Source: https://www.ema.europa.eu/en/medicines/human/orphan-designations/eu-3-25-3173